EMA orphan designation EU/3/19/2134
Active substance: 9-cis, 12-cis-11,11-D2-linoleic acid ethyl ester
Designated 2019-02-26. Orphan designation status: Positive.
First published: 2019-04-09.

Intended use: Treatment of infantile neuroaxonal dystrophy

Source: https://www.ema.europa.eu/en/medicines/human/orphan-designations/eu-3-19-2134